EMA orphan designation EU/3/25/3171
Active substance: puliretgene parvec
Designated 2025-09-12. Orphan designation status: Positive.
First published: 2026-03-26.

Intended use: Treatment of inherited retinal dystrophy due to dysfunction in the CYP4V2-gene

Source: https://www.ema.europa.eu/en/medicines/human/orphan-designations/eu-3-25-3171